EMA orphan designation EU/3/25/3185
Active substance: human IgG1 kappa monoclonal antibody against pappalysin-1
Designated 2026-01-09. Orphan designation status: Positive.
First published: 2026-03-25.

Intended use: Treatment of autosomal dominant polycystic kidney disease

Source: https://www.ema.europa.eu/en/medicines/human/orphan-designations/eu-3-25-3185-0